EMA orphan designation EU/3/12/957
Active substance: autologous haematopoietic cells genetically modified with a lentiviral vector containing the human gp91(phox) gene
Designated 2012-02-09. Orphan designation status: Positive.
First published: 2012-03-01. Last updated: 2024-10-11.

Intended use: Treatment of X-linked chronic granulomatous disease

Source: https://www.ema.europa.eu/en/medicines/human/orphan-designations/eu-3-12-957